EMA orphan designation EU/3/19/2155
Active substance: human culture expanded autologous mesenchymal stromal cells
Designated 2019-04-24. Orphan designation status: Positive.
First published: 2019-06-14. Last updated: 2022-05-03.

Intended use: Treatment of amyotrophic lateral sclerosis

Source: https://www.ema.europa.eu/en/medicines/human/orphan-designations/eu-3-19-2155